EMA orphan designation EU/3/19/2188: Kizfizo
Active substance: temozolomide
Designated 2019-08-21. Orphan designation status: Positive. EMA product number: EMEA/H/C/006169.
First published: 2020-01-14. Last updated: 2024-11-15.

Intended use: Treatment of neuroblastoma

Source: https://www.ema.europa.eu/en/medicines/human/orphan-designations/eu-3-19-2188